EMA orphan designation EU/3/13/1206
Active substance: synthetic 12 amino acid peptide designed after subcommissural organ-spondin
Designated 2013-11-13. Orphan designation status: Positive.
First published: 2013-12-02. Last updated: 2021-04-07.

Intended use: Treatment of spinal cord injury

Source: https://www.ema.europa.eu/en/medicines/human/orphan-designations/eu-3-13-1206